EMA orphan designation EU/3/08/540
Active substance: omigapil maleate
Designated 2008-05-08. Orphan designation status: Withdrawn.
First published: 2008-07-29. Last updated: 2025-01-15.

Intended use: Treatment of congenital muscular dystrophy with collagen VI deficiency

Source: https://www.ema.europa.eu/en/medicines/human/orphan-designations/eu-3-08-540